EMA orphan designation EU/3/12/995
Active substance: pegylated recombinant factor VIII (turoctocog alfa pegol)
Designated 2012-04-26. Orphan designation status: Withdrawn.
First published: 2012-06-18. Last updated: 2019-06-28.

Intended use: Treatment of haemophilia A

Source: https://www.ema.europa.eu/en/medicines/human/orphan-designations/eu-3-12-995